EMA orphan designation EU/3/22/2641
Active substance: eltanexor
Designated 2022-06-21. Orphan designation status: Positive.
First published: 2022-09-22. Last updated: 2026-05-11.

Intended use: Treatment of myelodysplastic syndromes

Source: https://www.ema.europa.eu/en/medicines/human/orphan-designations/eu-3-22-2641